EMA orphan designation EU/3/11/934
Active substance: 4-[[9-[(3S)-tetrahydro-3-furanyl]-8-[(2,4,6-trifluorophenyl)amino]-9H-purin-2-yl]amino]-trans-cyclohexanol (tanzisertib)
Designated 2011-12-09. Orphan designation status: Withdrawn.
First published: 2011-12-20. Last updated: 2018-02-22.

Intended use: Treatment of idiopathic pulmonary fibrosis

Source: https://www.ema.europa.eu/en/medicines/human/orphan-designations/eu-3-11-934